EMA orphan designation EU/3/18/2000
Active substance: recombinant human acid alpha-glucosidase
Designated 2018-03-21. Orphan designation status: Withdrawn.
First published: 2018-05-15. Last updated: 2023-05-30.

Intended use: Treatment of glycogen storage disease type II (Pompe's disease)

Source: https://www.ema.europa.eu/en/medicines/human/orphan-designations/eu-3-18-2000